EMA orphan designation EU/3/07/505
Active substance: Interferon beta
Designated 2007-11-29. Orphan designation status: Withdrawn.
First published: 2008-07-02. Last updated: 2024-04-11.

Intended use: Treatment of acute respiratory distress syndrome (ARDS)

Source: https://www.ema.europa.eu/en/medicines/human/orphan-designations/eu-3-07-505